EMA orphan designation EU/3/19/2187
Active substance: recombinant mutated extracellular domain of the human acetylcholine receptor subunit alpha1
Designated 2019-07-25. Orphan designation status: Positive.
First published: 2019-09-24.

Intended use: Treatment of myasthenia gravis

Source: https://www.ema.europa.eu/en/medicines/human/orphan-designations/eu-3-19-2187